EMA orphan designation EU/3/18/2032
Active substance: carmustine
Designated 2018-06-27. Orphan designation status: Positive.
First published: 2018-08-21. Last updated: 2018-08-21.

Intended use: Treatment in haematopoietic stem cell transplantation

Source: https://www.ema.europa.eu/en/medicines/human/orphan-designations/eu-3-18-2032